EMA orphan designation EU/3/23/2816
Active substance: rituximab
Designated 2023-07-25. Orphan designation status: Positive.
First published: 2024-01-31.

Intended use: Treatment of primary membranous nephropathy

Source: https://www.ema.europa.eu/en/medicines/human/orphan-designations/eu-3-23-2816